EMA orphan designation EU/3/17/1921
Active substance: cannabidivarin
Designated 2017-10-16. Orphan designation status: Positive.
First published: 2018-01-08. Last updated: 2023-06-15.

Intended use: Treatment of Rett syndrome

Source: https://www.ema.europa.eu/en/medicines/human/orphan-designations/eu-3-17-1921